EMA orphan designation EU/3/13/1195
Active substance: antisense oligonucleotide targeting the F508delta mutation of CFTR (eluforsen)
Designated 2013-10-07. Orphan designation status: Withdrawn.
First published: 2013-10-31. Last updated: 2020-12-18.

Intended use: Treatment of cystic fibrosis

Source: https://www.ema.europa.eu/en/medicines/human/orphan-designations/eu-3-13-1195